EMA orphan designation EU/3/04/197: Tyvaso
Active substance: treprostinil sodium
Designated 2004-04-14. Orphan designation status: Positive. EMA product number: EMEA/H/C/001115.
First published: 2009-09-23. Last updated: 2023-02-13.

Intended use: Treatment of pulmonary arterial hypertension and chronic thromboembolic pulmonary hypertension

Source: https://www.ema.europa.eu/en/medicines/human/orphan-designations/eu-3-04-197